EMA orphan designation EU/3/23/2804
Active substance: omadacycline
Orphan designation status: Positive.
First published: 2024-02-01.

Intended use: Treatment of nontuberculous mycobacterial lung disease

Source: https://www.ema.europa.eu/en/medicines/human/orphan-designations/eu-3-23-2804